EMA orphan designation EU/3/04/246
Active substance: valproic acid
Designated 2004-11-30. Orphan designation status: Withdrawn.
First published: 2006-01-04. Last updated: 2014-01-13.

Intended use: Treatment of familial adenomatous polyposis (FAP)

Source: https://www.ema.europa.eu/en/medicines/human/orphan-designations/eu-3-04-246